EMA orphan designation EU/3/21/2558
Active substance: mRNA encoding human glucose-6-phosphatase variant S298C
Designated 2022-01-14. Orphan designation status: Positive.
First published: 2022-06-09.

Intended use: treatment of glycogen storage disease type Ia

Source: https://www.ema.europa.eu/en/medicines/human/orphan-designations/eu-3-21-2558